EMA orphan designation EU/3/20/2347
Active substance: trehalose
Designated 2020-10-19. Orphan designation status: Withdrawn.
First published: 2021-02-16. Last updated: 2025-03-12.

Intended use: Treatment of neuronal ceroid lipofuscinosis

Source: https://www.ema.europa.eu/en/medicines/human/orphan-designations/eu-3-20-2347